EMA orphan designation EU/3/10/799
Active substance: tecovirimat
Designated 2010-10-01. Orphan designation status: Withdrawn.
First published: 2010-10-14. Last updated: 2012-03-28.

Intended use: Treatment of cowpox infection

Source: https://www.ema.europa.eu/en/medicines/human/orphan-designations/eu-3-10-799